EMA orphan designation EU/3/18/2132
Active substance: synthetic double-stranded siRNA oligonucleotide directed against TMPRSS6 mRNA and covalently linked to a ligand containing three N-acetylgalactosamine residues
Designated 2019-01-11. Orphan designation status: Withdrawn.
First published: 2019-03-27.

Intended use: Treatment of beta thalassaemia intermedia and major

Source: https://www.ema.europa.eu/en/medicines/human/orphan-designations/eu-3-18-2132